EMA orphan designation EU/3/13/1229
Active substance: autologous dendritic cells pulsed with allogeneic tumour cell lysate
Designated 2014-01-16. Orphan designation status: Positive.
First published: 2014-03-24. Last updated: 2015-03-23.

Intended use: Treatment of malignant mesothelioma

Source: https://www.ema.europa.eu/en/medicines/human/orphan-designations/eu-3-13-1229